EMA orphan designation EU/3/25/3160
Active substance: adeno-associated virus vector serotype 6.2 containing human TERT gene
Designated 2025-09-12. Orphan designation status: Positive.
First published: 2026-03-26.

Intended use: Treatment of idiopathic pulmonary fibrosis

Source: https://www.ema.europa.eu/en/medicines/human/orphan-designations/eu-3-25-3160